EMA orphan designation EU/3/15/1540
Active substance: adeno-associated viral vector serotype 9 containing the human iduronate-2-sulfatase gene
Designated 2015-08-10. Orphan designation status: Withdrawn.
First published: 2015-08-10. Last updated: 2025-02-20.

Intended use: Treatment of mucopolysaccharidosis type II (Hunter's syndrome)

Source: https://www.ema.europa.eu/en/medicines/human/orphan-designations/eu-3-15-1540